EMA orphan designation EU/3/24/2939
Active substance: 2-(((2E)-3-(3-methoxy-4-(2-propyn-1-yloxy)phenyl)-1-oxo-2-propen-1-yl)amino)benzoic acid
Designated 2024-06-28. Orphan designation status: Positive.
First published: 2024-08-05.

Intended use: Treatment of systemic sclerosis

Source: https://www.ema.europa.eu/en/medicines/human/orphan-designations/eu-3-24-2939